EMA orphan designation EU/3/06/423
Active substance: tazarotene
Designated 2006-12-18. Orphan designation status: Withdrawn.
First published: 2009-04-02. Last updated: 2015-03-06.

Intended use: Treatment of congenital ichthyoses

Source: https://www.ema.europa.eu/en/medicines/human/orphan-designations/eu-3-06-423